EMA orphan designation EU/3/18/2110
Active substance: afatinib
Designated 2018-12-14. Orphan designation status: Positive.
First published: 2019-03-08. Last updated: 2022-11-11.

Intended use: Treatment of Fanconi anaemia

Source: https://www.ema.europa.eu/en/medicines/human/orphan-designations/eu-3-18-2110